EMA orphan designation EU/3/05/325
Active substance: Mannitolum
Designated 2005-11-07. Orphan designation status: Expired.
First published: 2008-07-29. Last updated: 2022-05-05.

Intended use: Treatment of cystic fibrosis

Source: https://www.ema.europa.eu/en/medicines/human/orphan-designations/eu-3-05-325